EMA orphan designation EU/3/19/2190
Active substance: peginterferon lambda-1a
Designated 2019-08-21. Orphan designation status: Positive.
First published: 2020-01-14.

Intended use: Treatment of hepatitis D virus infection

Source: https://www.ema.europa.eu/en/medicines/human/orphan-designations/eu-3-19-2190